EMA orphan designation EU/3/13/1113
Active substance: murine IgM monoclonal antibody binding to alpha beta T-cell receptor
Designated 2013-03-12. Orphan designation status: Withdrawn.
First published: 2013-04-05. Last updated: 2017-07-17.

Intended use: Prevention of graft rejection following solid organ transplantation

Source: https://www.ema.europa.eu/en/medicines/human/orphan-designations/eu-3-13-1113